EMA orphan designation EU/3/10/836
Active substance: paquinimod
Designated 2011-02-23. Orphan designation status: Positive.
First published: 2011-03-07. Last updated: 2011-03-07.

Intended use: Treatment of systemic sclerosis

Source: https://www.ema.europa.eu/en/medicines/human/orphan-designations/eu-3-10-836